EMA orphan designation EU/3/10/819: Heparesc
Active substance: human heterologous liver cells
Designated 2010-12-17. Orphan designation status: Positive. EMA product number: EMEA/H/C/003750.
First published: 2011-01-19. Last updated: 2016-08-16.

Intended use: Treatment of hyperargininaemia

Source: https://www.ema.europa.eu/en/medicines/human/orphan-designations/eu-3-10-819